EMA orphan designation EU/3/12/1049
Active substance: rucaparib
Designated 2012-10-10. Orphan designation status: Withdrawn.
First published: 2012-11-16. Last updated: 2019-02-19.

Intended use: Treatment of ovarian cancer

Source: https://www.ema.europa.eu/en/medicines/human/orphan-designations/eu-3-12-1049